EMA orphan designation EU/3/17/1941: Viokat
Active substance: diazoxide choline
Designated 2017-10-12. Orphan designation status: Positive. EMA product number: EMEA/H/C/006576.
First published: 2018-01-12. Last updated: 2026-04-24.

Intended use: Treatment of Prader-Willi syndrome

Source: https://www.ema.europa.eu/en/medicines/human/orphan-designations/eu-3-17-1941